EMA orphan designation EU/3/13/1201
Active substance: defibrotide
Designated 2013-11-13. Orphan designation status: Withdrawn.
First published: 2013-12-02. Last updated: 2025-06-05.

Intended use: Prevention of graft-versus-host disease

Source: https://www.ema.europa.eu/en/medicines/human/orphan-designations/eu-3-13-1201